EMA orphan designation EU/3/25/3157
Active substance: Infigratinib
Designated 2025-11-21. Orphan designation status: Positive.
First published: 2026-04-14.

Intended use: Treatment of hypochondroplasia

Source: https://www.ema.europa.eu/en/medicines/human/orphan-designations/eu-3-25-3157